EMA orphan designation EU/3/14/1336
Active substance: (S)-6-hydroxy-2,5,7,8-tetramethyl-N-((R)-piperidin-3-yl)chroman-2-carboxamide hydrochloride
Designated 2014-10-15. Orphan designation status: Withdrawn.
First published: 2014-11-12. Last updated: 2023-03-27.

Intended use: Treatment of Leigh syndrome

Source: https://www.ema.europa.eu/en/medicines/human/orphan-designations/eu-3-14-1336